EMA orphan designation EU/3/06/391
Active substance: amphotericin B
Designated 2006-08-28. Orphan designation status: Withdrawn.
First published: 2009-04-02. Last updated: 2019-02-21.

Intended use: Prevention of pulmonary fungal infection in patients deemed at risk

Source: https://www.ema.europa.eu/en/medicines/human/orphan-designations/eu-3-06-391